EMA orphan designation EU/3/24/3024
Active substance: clofutriben
Designated 2025-01-16. Orphan designation status: Positive.
First published: 2025-02-20.

Intended use: Treatment of Cushing's syndrome of endogenous origin

Source: https://www.ema.europa.eu/en/medicines/human/orphan-designations/eu-3-24-3024